EMA orphan designation EU/3/05/283
Active substance: recombinant megakaryopoiesis-stimulating protein
Designated 2005-05-27. Orphan designation status: Expired.
First published: 2005-06-29. Last updated: 2019-02-20.

Intended use: Treatment of idiopathic thrombocytopenic purpura

Source: https://www.ema.europa.eu/en/medicines/human/orphan-designations/eu-3-05-283